EMA orphan designation EU/3/11/850
Active substance: Darinaparsin
Designated 2011-04-15. Orphan designation status: Positive.
First published: 2011-04-26. Last updated: 2022-07-14.

Intended use: Treatment of peripheral T-cell lymphoma (nodal, other extranodal and leukaemic/disseminated)

Source: https://www.ema.europa.eu/en/medicines/human/orphan-designations/eu-3-11-850